EMA orphan designation EU/3/21/2461
Active substance: 1-(4-(6-chloropyridazin-3-yl)piperazin-1-yl)-2-(4-cyclopropyl-3-fluorophenyl)ethan-1-one
Designated 2021-07-19. Orphan designation status: Withdrawn.
First published: 2021-11-12. Last updated: 2025-06-10.

Intended use: Treatment of pantothenate kinase-associated neurodegeneration

Source: https://www.ema.europa.eu/en/medicines/human/orphan-designations/eu-3-21-2461